EMA orphan designation EU/3/19/2164
Active substance: relacorilant
Designated 2019-05-29. Orphan designation status: Positive.
First published: 2019-08-09. Last updated: 2024-10-22.

Intended use: Treatment of Cushing's syndrome

Source: https://www.ema.europa.eu/en/medicines/human/orphan-designations/eu-3-19-2164